EMA orphan designation EU/3/21/2511
Active substance: adeno-associated virus serotype rh10 containing the human GALC gene
Designated 2021-10-15. Orphan designation status: Positive.
First published: 2022-01-21. Last updated: 2024-04-08.

Intended use: Treatment of Krabbe disease

Source: https://www.ema.europa.eu/en/medicines/human/orphan-designations/eu-3-21-2511